EMA orphan designation EU/3/15/1532
Active substance: insulin human
Designated 2015-08-10. Orphan designation status: Positive.
First published: 2015-10-02. Last updated: 2015-10-02.

Intended use: Treatment of short bowel syndrome

Source: https://www.ema.europa.eu/en/medicines/human/orphan-designations/eu-3-15-1532